EMA orphan designation EU/3/22/2672
Active substance: toll-like receptor 4 agonist
Designated 2022-08-10. Orphan designation status: Positive.
First published: 2022-12-15.

Intended use: Treatment of osteosarcoma

Source: https://www.ema.europa.eu/en/medicines/human/orphan-designations/eu-3-22-2672